EMA orphan designation EU/3/11/869
Active substance: Lisuride hydrogen maleate
Designated 2011-05-13. Orphan designation status: Positive.
First published: 2011-05-31. Last updated: 2013-04-09.

Intended use: Treatment of pulmonary arterial hypertension and chronic thromboembolic pulmonary hypertension

Source: https://www.ema.europa.eu/en/medicines/human/orphan-designations/eu-3-11-869